EMA orphan designation EU/3/12/1027
Active substance: human apotransferrin
Designated 2012-07-17. Orphan designation status: Positive.
First published: 2012-08-31. Last updated: 2016-08-15.

Intended use: Treatment of congenital hypotransferrinaemia

Source: https://www.ema.europa.eu/en/medicines/human/orphan-designations/eu-3-12-1027